EMA orphan designation EU/3/22/2581
Active substance: Epcoritamab
Designated 2022-02-24. Orphan designation status: Withdrawn.
First published: 2022-05-13.

Intended use: Treatment of diffuse large B-cell lymphoma

Source: https://www.ema.europa.eu/en/medicines/human/orphan-designations/eu-3-22-2581